EMA orphan designation EU/3/14/1263
Active substance: autologous CD34+ haematopoietic stem cells transduced with lentiviral vector encoding the human beta A-T87Q-globin gene
Designated 2014-04-29. Orphan designation status: Withdrawn.
First published: 2014-06-04. Last updated: 2021-12-07.

Intended use: The treatment of sickle cell disease

Source: https://www.ema.europa.eu/en/medicines/human/orphan-designations/eu-3-14-1263